EMA orphan designation EU/3/26/3195
Active substance: sefaxersen
Designated 2026-02-23. Orphan designation status: Positive.
First published: 2026-04-14.

Intended use: Treatment of primary IgA nephropathy

Source: https://www.ema.europa.eu/en/medicines/human/orphan-designations/eu-3-26-3195